EMA orphan designation EU/3/15/1514
Active substance: Lanreotide acetate
Designated 2015-08-10. Orphan designation status: Positive.
First published: 2015-08-18. Last updated: 2015-08-18.

Intended use: Treatment of autosomal dominant polycystic kidney disease

Source: https://www.ema.europa.eu/en/medicines/human/orphan-designations/eu-3-15-1514